EMA orphan designation EU/3/10/733: Ravicti
Active substance: Glyceryl tri-(4-phenylbutyrate)
Designated 2010-02-03. Orphan designation status: Positive. EMA product number: EMEA/H/C/003822.
First published: 2010-06-24. Last updated: 2022-11-18.

Intended use: Treatment of carbamoyl-phosphate synthase-1 deficiency

Source: https://www.ema.europa.eu/en/medicines/human/orphan-designations/eu-3-10-733